EMA orphan designation EU/3/18/1977
Active substance: cannabidivarin
Designated 2018-02-22. Orphan designation status: Positive.
First published: 2018-04-11. Last updated: 2023-06-15.

Intended use: Treatment of fragile X syndrome

Source: https://www.ema.europa.eu/en/medicines/human/orphan-designations/eu-3-18-1977